EMA orphan designation EU/3/12/1015
Active substance: Eculizumab
Designated 2012-07-04. Orphan designation status: Withdrawn.
First published: 2012-07-24. Last updated: 2016-01-19.

Intended use: Treatment of infection-associated haemolytic uraemic syndrome

Source: https://www.ema.europa.eu/en/medicines/human/orphan-designations/eu-3-12-1015